EMA orphan designation EU/3/13/1157
Active substance: (S)-3-(1-(9H-purin-6-ylamino)ethyl)-8-chloro-2-phenylisoquinolin-1(2H)-one
Designated 2013-07-17. Orphan designation status: Positive.
First published: 2013-08-16. Last updated: 2021-05-31.

Intended use: Treatment of follicular lymphoma

Source: https://www.ema.europa.eu/en/medicines/human/orphan-designations/eu-3-13-1157